EMA orphan designation EU/3/12/971
Active substance: heterologous human adult liver-derived stem cells
Designated 2012-03-05. Orphan designation status: Positive.
First published: 2012-04-19. Last updated: 2021-08-26.

Intended use: Treatment of carbamoyl-phosphate synthase-1 deficiency

Source: https://www.ema.europa.eu/en/medicines/human/orphan-designations/eu-3-12-971